EMA orphan designation EU/3/19/2143: Zepzelca
Active substance: lurbinectedin
Designated 2019-02-26. Orphan designation status: Positive. EMA product number: EMEA/H/C/006673.
First published: 2019-04-09. Last updated: 2026-03-27.

Intended use: Treatment of small cell lung cancer

Source: https://www.ema.europa.eu/en/medicines/human/orphan-designations/eu-3-19-2143